EMA orphan designation EU/3/07/514: Cerdelga
Designated 2007-12-04. Orphan designation status: Positive. EMA product number: EMEA/H/C/003724.
First published: 2008-07-02. Last updated: 2023-07-18.

Intended use: Treatment of Gaucher disease

Source: https://www.ema.europa.eu/en/medicines/human/orphan-designations/eu-3-07-514